EMA orphan designation EU/3/16/1823
Active substance: pioglitazone hydrochloride
Designated 2017-01-12. Orphan designation status: Positive.
First published: 2017-07-11. Last updated: 2017-07-11.

Intended use: Treatment of sudden sensorineural hearing loss

Source: https://www.ema.europa.eu/en/medicines/human/orphan-designations/eu-3-16-1823